EMA orphan designation EU/3/16/1628
Active substance: Diaspirin cross-linked haemoglobin
Designated 2016-03-21. Orphan designation status: Positive.
First published: 2016-05-02. Last updated: 2019-09-30.

Intended use: Treatment of oesophageal cancer

Source: https://www.ema.europa.eu/en/medicines/human/orphan-designations/eu-3-16-1628